EMA orphan designation EU/3/09/685
Active substance: N-[(2S)-2,3-dihydroxypropyl]-3-[(2-fluoro-4-iodophenyl)amino]isonicotinamide hydrochloride
Designated 2009-10-08. Orphan designation status: Withdrawn.
First published: 2009-11-19. Last updated: 2020-08-12.

Intended use: Treatment of pancreatic cancer

Source: https://www.ema.europa.eu/en/medicines/human/orphan-designations/eu-3-09-685